EMA orphan designation EU/3/18/2079
Active substance: recombinant adeno-associated viral vector containing a bioengineered capsid and a codon-optimised expression cassette to drive the expression of the SQ form of a B-domain deleted human coagulation factor VIII
Designated 2018-10-26. Orphan designation status: Withdrawn.
First published: 2019-01-10. Last updated: 2019-04-24.

Intended use: Treatment of haemophilia A

Source: https://www.ema.europa.eu/en/medicines/human/orphan-designations/eu-3-18-2079